EMA orphan designation EU/3/12/963: Ledaga
Active substance: chlormethine
Designated 2012-05-22. Orphan designation status: Positive. EMA product number: EMEA/H/C/002826.
First published: 2012-07-06. Last updated: 2017-03-16.

Intended use: Treatment of cutaneous T-cell lymphoma

Source: https://www.ema.europa.eu/en/medicines/human/orphan-designations/eu-3-12-963